EMA orphan designation EU/3/04/198
Active substance: human monoclonal antibody against CD4
Designated 2004-04-14. Orphan designation status: Positive.
First published: 2008-08-18. Last updated: 2011-03-10.

Intended use: Treatment of cutaneous T-cell lymphoma

Source: https://www.ema.europa.eu/en/medicines/human/orphan-designations/eu-3-04-198